EMA orphan designation EU/3/08/572
Active substance: (R)-3-(4-(7H-pyrrolo[2,3-d]pyrimidin-4-yl)-1H-pyrazol-1-yl)-3-cyclopentylpropanenitrile phosphate
Designated 2008-11-07. Orphan designation status: Withdrawn.
First published: 2009-06-29. Last updated: 2015-05-08.

Intended use: Treatment of chronic idiopathic myelofibrosis

Source: https://www.ema.europa.eu/en/medicines/human/orphan-designations/eu-3-08-572